EMA orphan designation EU/3/06/406
Active substance: arimoclomol
Orphan designation status: Withdrawn.
First published: 2009-04-02. Last updated: 2022-07-19.

Intended use: Treatment of amyotrophic lateral sclerosis

Source: https://www.ema.europa.eu/en/medicines/human/orphan-designations/eu-3-06-406